EMA orphan designation EU/3/21/2486: Talvey
Active substance: talquetamab
Designated 2021-08-20. Orphan designation status: Positive. EMA product number: EMEA/H/C/005864.
First published: 2022-02-25. Last updated: 2023-09-21.

Intended use: Treatment of multiple myeloma

Source: https://www.ema.europa.eu/en/medicines/human/orphan-designations/eu-3-21-2486